EMA orphan designation EU/3/17/1858
Active substance: Megestrol acetate
Designated 2017-03-20. Orphan designation status: Positive.
First published: 2017-05-05. Last updated: 2017-05-05.

Intended use: Treatment of granulosa cell tumours

Source: https://www.ema.europa.eu/en/medicines/human/orphan-designations/eu-3-17-1858